EMA orphan designation EU/3/19/2150
Active substance: codon-optimised human cystic fibrosis transmembrane conductance regulator messenger ribonucleic acid complexed with lipid-based nanoparticles; pomulmeran
Designated 2019-04-01. Orphan designation status: Withdrawn.
First published: 2019-05-07. Last updated: 2023-07-21.

Intended use: Treatment of cystic fibrosis

Source: https://www.ema.europa.eu/en/medicines/human/orphan-designations/eu-3-19-2150